EMA orphan designation EU/3/10/756
Active substance: tranilast
Designated 2010-07-27. Orphan designation status: Positive.
First published: 2010-08-06. Last updated: 2024-04-11.

Intended use: Prevention of scarring post glaucoma filtration surgery

Source: https://www.ema.europa.eu/en/medicines/human/orphan-designations/eu-3-10-756